EMA orphan designation EU/3/24/2940
Active substance: nizubaglustat
Designated 2024-06-28. Orphan designation status: Positive.
First published: 2024-08-22.

Intended use: Treatment of GM1 gangliosidosis

Source: https://www.ema.europa.eu/en/medicines/human/orphan-designations/eu-3-24-2940